EMA orphan designation EU/3/22/2650
Active substance: zilucoplan
Designated 2022-07-18. Orphan designation status: Withdrawn.
First published: 2023-01-25. Last updated: 2026-05-26.

Intended use: Treatment of myasthenia gravis

Source: https://www.ema.europa.eu/en/medicines/human/orphan-designations/eu-3-22-2650